EMA orphan designation EU/3/10/845: Filsuvez
Active substance: dry extract from birch bark (DER 5-10 : 1), extraction solvent n-heptane 95% (w/w) (birch bark extract)
Designated 2011-02-23. Orphan designation status: Positive. EMA product number: EMEA/H/C/005035.
First published: 2011-03-04. Last updated: 2024-10-22.

Intended use: Treatment of epidermolysis bullosa

Source: https://www.ema.europa.eu/en/medicines/human/orphan-designations/eu-3-10-845